EMA orphan designation EU/3/25/3123
Active substance: leniolisib
Designated 2025-08-22. Orphan designation status: Positive.
First published: 2026-04-10.

Intended use: Treatment of common variable immunodeficiency (CVID)

Source: https://www.ema.europa.eu/en/medicines/human/orphan-designations/eu-3-25-3123